EMA orphan designation EU/3/18/2099
Active substance: lisocabtagene maraleucel
Designated 2018-11-19. Orphan designation status: Withdrawn.
First published: 2019-02-25. Last updated: 2022-05-04.

Intended use: Treatment of primary mediastinal large B-cell lymphoma

Source: https://www.ema.europa.eu/en/medicines/human/orphan-designations/eu-3-18-2099